EMA orphan designation EU/3/17/1960
Active substance: ciclopirox
Designated 2018-01-17. Orphan designation status: Positive.
First published: 2018-03-14. Last updated: 2018-03-14.

Intended use: Treatment of congenital erythropoietic porphyria

Source: https://www.ema.europa.eu/en/medicines/human/orphan-designations/eu-3-17-1960